EMA orphan designation EU/3/02/131
Active substance: sodium oxybate
Designated 2003-02-03. Orphan designation status: Withdrawn.
First published: 2007-02-28. Last updated: 2010-02-22.

Intended use: Treatment of narcolepsy

Source: https://www.ema.europa.eu/en/medicines/human/orphan-designations/eu-3-02-131